EMA orphan designation EU/3/19/2246
Active substance: romilkimab
Designated 2020-01-09. Orphan designation status: Withdrawn.
First published: 2020-05-20. Last updated: 2021-04-06.

Intended use: Treatment of systemic sclerosis

Source: https://www.ema.europa.eu/en/medicines/human/orphan-designations/eu-3-19-2246